EMA orphan designation EU/3/10/763
Active substance: dexamethasone
Designated 2010-08-04. Orphan designation status: Withdrawn.
First published: 2010-08-11. Last updated: 2010-11-19.

Intended use: Treatment of non-infectious uveitis affecting the posterior segment of the eye

Source: https://www.ema.europa.eu/en/medicines/human/orphan-designations/eu-3-10-763